EMA orphan designation EU/3/23/2780
Active substance: adeno-associated virus serotype 9 expressing a transcription factor for the SCN1A gene
Designated 2023-05-22. Orphan designation status: Positive.
First published: 2024-01-24.

Intended use: Treatment of Dravet syndrome

Source: https://www.ema.europa.eu/en/medicines/human/orphan-designations/eu-3-23-2780